EMA orphan designation EU/3/07/500
Active substance: recombinant human rod-derived cone viability factor
Designated 2007-11-29. Orphan designation status: Withdrawn.
First published: 2009-09-23. Last updated: 2015-03-06.

Intended use: Treatment of retinitis pigmentosa

Source: https://www.ema.europa.eu/en/medicines/human/orphan-designations/eu-3-07-500